EMA orphan designation EU/3/07/437: Puldysa
Active substance: idebenone
Designated 2007-03-20. Orphan designation status: Positive. EMA product number: EMEA/H/C/005123.
First published: 2007-07-19. Last updated: 2024-10-22.

Intended use: Treatment of Duchenne muscular dystrophy

Source: https://www.ema.europa.eu/en/medicines/human/orphan-designations/eu-3-07-437